EMA orphan designation EU/3/13/1170
Active substance: octreotide acetate
Designated 2013-08-05. Orphan designation status: Withdrawn.
First published: 2013-09-12. Last updated: 2025-03-21.

Intended use: Treatment of acromegaly

Source: https://www.ema.europa.eu/en/medicines/human/orphan-designations/eu-3-13-1170